EMA orphan designation EU/3/18/2084
Active substance: anetumab ravtansine
Designated 2018-11-19. Orphan designation status: Withdrawn.
First published: 2019-02-25. Last updated: 2022-02-01.

Intended use: Treatment of ovarian cancer

Source: https://www.ema.europa.eu/en/medicines/human/orphan-designations/eu-3-18-2084